EMA orphan designation EU/3/21/2399
Active substance: 18-(p-[131I]-iodophenyl)octadecyl phosphocholine
Designated 2021-02-19. Orphan designation status: Positive.
First published: 2022-02-22.

Intended use: Treatment of lymphoplasmacytic lymphoma

Source: https://www.ema.europa.eu/en/medicines/human/orphan-designations/eu-3-21-2399